EMA orphan designation EU/3/13/1100
Active substance: Humanised IgG1 kappa antibody against serum amyloid A and AL amyloid
Designated 2013-02-08. Orphan designation status: Positive.
First published: 2013-03-04. Last updated: 2024-10-11.

Intended use: Treatment of amyloid light-chain amyloidosis

Source: https://www.ema.europa.eu/en/medicines/human/orphan-designations/eu-3-13-1100